EMA orphan designation EU/3/21/2494
Active substance: sabatolimab
Designated 2021-08-20. Orphan designation status: Withdrawn.
First published: 2022-03-02. Last updated: 2026-05-26.

Intended use: Treatment of myelodysplastic syndromes

Source: https://www.ema.europa.eu/en/medicines/human/orphan-designations/eu-3-21-2494